EMA orphan designation EU/3/15/1553: Yescarta
Active substance: autologous T cells transduced with retroviral vector encoding an anti-CD19 CD28/CD3-zeta chimeric antigen receptor (axicabtagene ciloleucel)
Designated 2015-10-09. Orphan designation status: Positive. EMA product number: EMEA/H/C/004480.
First published: 2015-11-16. Last updated: 2021-09-15.

Intended use: Treatment of primary mediastinal large B-cell lymphoma

Source: https://www.ema.europa.eu/en/medicines/human/orphan-designations/eu-3-15-1553